EMA orphan designation EU/3/13/1147
Active substance: Granulocyte-macrophage colony-stimulating factor
Designated 2013-07-17. Orphan designation status: Positive.
First published: 2013-08-21. Last updated: 2022-04-06.

Intended use: Treatment of pulmonary alveolar proteinosis

Source: https://www.ema.europa.eu/en/medicines/human/orphan-designations/eu-3-13-1147